EMA orphan designation EU/3/14/1251
Active substance: recombinant human alpha-glucosidase conjugated with multiple copies of synthetic bismannose-6-phosphate-tetra-mannose glycan (avalglucosidase alfa)
Designated 2014-03-26. Orphan designation status: Withdrawn.
First published: 2014-05-06. Last updated: 2022-08-02.

Intended use: Treatment of glycogen storage disease type II (Pompe's disease)

Source: https://www.ema.europa.eu/en/medicines/human/orphan-designations/eu-3-14-1251